EMA orphan designation EU/3/23/2860
Active substance: allogeneic amniotic fluid-derived mesenchymal stem cells with lung specificity
Designated 2023-12-13. Orphan designation status: Positive.
First published: 2024-02-01.

Intended use: Prevention of primary graft dysfunction following lung transplantation

Source: https://www.ema.europa.eu/en/medicines/human/orphan-designations/eu-3-23-2860